EMA orphan designation EU/3/15/1433
Active substance: sevuparin sodium
Designated 2015-02-12. Orphan designation status: Positive.
First published: 2015-04-08. Last updated: 2017-03-17.

Intended use: Treatment of sickle cell disease

Source: https://www.ema.europa.eu/en/medicines/human/orphan-designations/eu-3-15-1433